EMA orphan designation EU/3/07/443
Active substance: Elafin
Designated 2007-03-20. Orphan designation status: Positive.
First published: 2008-07-02. Last updated: 2023-06-20.

Intended use: Treatment of pulmonary arterial hypertension and chronic thromboembolic pulmonary hypertension

Source: https://www.ema.europa.eu/en/medicines/human/orphan-designations/eu-3-07-443